EMA orphan designation EU/3/24/2955
Active substance: heterologous swine glyco-humanised polyclonal antibody against T lymphocytes
Designated 2024-07-25. Orphan designation status: Positive.
First published: 2024-09-13.

Intended use: Treatment of peripheral T-cell lymphoma

Source: https://www.ema.europa.eu/en/medicines/human/orphan-designations/eu-3-24-2955